EMA orphan designation EU/3/13/1165
Active substance: heterologous human adult liver-derived progenitor cells
Designated 2013-07-17. Orphan designation status: Positive.
First published: 2013-08-22. Last updated: 2024-10-15.

Intended use: Treatment of N-acetylglutamate synthetase (NAGS) deficiency

Source: https://www.ema.europa.eu/en/medicines/human/orphan-designations/eu-3-13-1165